EMA orphan designation EU/3/17/1879
Active substance: Asp-Arg-Val-Tyr-Ile-His-Pro (Angiotensin 1-7); talfirastide
Designated 2017-06-20. Orphan designation status: Positive.
First published: 2017-07-17. Last updated: 2024-10-22.

Intended use: Treatment of epidermolysis bullosa

Source: https://www.ema.europa.eu/en/medicines/human/orphan-designations/eu-3-17-1879